EMA orphan designation EU/3/12/1066: Vyndaqel
Active substance: tafamidis
Designated 2012-11-08. Orphan designation status: Positive. EMA product number: EMEA/H/C/002294.
First published: 2012-12-13. Last updated: 2020-02-25.

Intended use: Treatment of senile systemic amyloidosis

Source: https://www.ema.europa.eu/en/medicines/human/orphan-designations/eu-3-12-1066